EMA orphan designation EU/3/08/555
Active substance: vincristine sulfate liposomes
Designated 2008-07-08. Orphan designation status: Withdrawn.
First published: 2009-06-29. Last updated: 2026-05-26.

Intended use: Treatment of acute lymphoblastic leukaemia

Source: https://www.ema.europa.eu/en/medicines/human/orphan-designations/eu-3-08-555